EMA orphan designation EU/3/18/2121
Active substance: sodium 2-hydroxylinoleate
Designated 2018-12-14. Orphan designation status: Positive.
First published: 2019-03-08.

Intended use: Treatment of biliary tract cancer

Source: https://www.ema.europa.eu/en/medicines/human/orphan-designations/eu-3-18-2121